EMA orphan designation EU/3/00/002
Active substance: alpha-galactosidase A
Designated 2000-08-08. Orphan designation status: Expired.
First published: 2010-10-18. Last updated: 2011-11-18.

Intended use: Treatment of Fabry disease

Source: https://www.ema.europa.eu/en/medicines/human/orphan-designations/eu-3-00-002